EMA orphan designation EU/3/26/3213
Active substance: telisotuzumab adizutecan
Designated 2026-03-25. Orphan designation status: Positive.
First published: 2026-04-22.

Intended use: Treatment of pancreatic cancer

Source: https://www.ema.europa.eu/en/medicines/human/orphan-designations/eu-3-26-3213